EMA orphan designation EU/3/03/164
Active substance: alpha-1-acid glycoprotein
Orphan designation status: Withdrawn.
First published: 2004-04-26. Last updated: 2004-04-26.

Intended use: Treatment of cocaine poisoning

Source: https://www.ema.europa.eu/en/medicines/human/orphan-designations/eu-3-03-164